EMA orphan designation EU/3/08/564
Active substance: avian polyclonal IgY antibody
Designated 2008-09-23. Orphan designation status: Positive.
First published: 2009-04-24. Last updated: 2009-04-24.

Intended use: Treatment of cystic fibrosis

Source: https://www.ema.europa.eu/en/medicines/human/orphan-designations/eu-3-08-564